EMA orphan designation EU/3/15/1506
Active substance: antisense oligonucleotide directed against TGF-β2 mRNA
Designated 2015-06-19. Orphan designation status: Positive.
First published: 2015-07-27. Last updated: 2015-07-27.

Intended use: Prevention of scarring post glaucoma filtration surgery

Source: https://www.ema.europa.eu/en/medicines/human/orphan-designations/eu-3-15-1506